EMA orphan designation EU/3/18/2131
Active substance: rozanolixizumab
Designated 2019-01-11. Orphan designation status: Withdrawn.
First published: 2019-03-27. Last updated: 2023-05-08.

Intended use: Treatment of immune thrombocytopenia

Source: https://www.ema.europa.eu/en/medicines/human/orphan-designations/eu-3-18-2131